EMA orphan designation EU/3/18/2061
Active substance: autologous glioma tumour cells treated with antisense molecule directed against the insulin-like growth factor type 1 receptor
Designated 2018-08-24. Orphan designation status: Positive.
First published: 2018-12-19.

Intended use: Treatment of glioma

Source: https://www.ema.europa.eu/en/medicines/human/orphan-designations/eu-3-18-2061